EMA orphan designation EU/3/14/1265
Active substance: genetically modified serotype 5/3 adenovirus coding for granulocyte macrophage colony-stimulating factor for the treatment of ovarian cancer
Designated 2014-04-29. Orphan designation status: Withdrawn.
First published: 2014-06-04. Last updated: 2024-04-09.

Intended use: Treatment of ovarian cancer

Source: https://www.ema.europa.eu/en/medicines/human/orphan-designations/eu-3-14-1265